EMA orphan designation EU/3/15/1437
Active substance: alvocidib
Designated 2015-02-12. Orphan designation status: Positive.
First published: 2015-04-09. Last updated: 2022-03-07.

Intended use: Treatment of acute myeloid leukaemia

Source: https://www.ema.europa.eu/en/medicines/human/orphan-designations/eu-3-15-1437